EMA orphan designation EU/3/15/1439
Active substance: allogeneic CD4+ and CD8+ T lymphocytes ex vivo incubated with synthetic peptides of the viral antigens of cytomegalovirus, adenovirus and Epstein-Barr virus
Designated 2015-02-12. Orphan designation status: Positive.
First published: 2015-04-09. Last updated: 2015-04-09.

Intended use: Treatment of cytomegalovirus infection following haematopoietic stem cell transplantation

Source: https://www.ema.europa.eu/en/medicines/human/orphan-designations/eu-3-15-1439